EMA orphan designation EU/3/20/2355
Active substance: anti-(pancreatic adenocarcinoma upregulated factor) IgG1 humanised monoclonal antibody
Designated 2020-11-13. Orphan designation status: Positive.
First published: 2021-02-15.

Intended use: Treatment of pancreatic cancer

Source: https://www.ema.europa.eu/en/medicines/human/orphan-designations/eu-3-20-2355